EMA orphan designation EU/3/03/136
Active substance: Iodine (131I) tositumomab
Designated 2003-02-14. Orphan designation status: Withdrawn.
First published: 2005-12-12. Last updated: 2015-05-08.

Intended use: Treatment of follicular lymphoma

Source: https://www.ema.europa.eu/en/medicines/human/orphan-designations/eu-3-03-136